EMA orphan designation EU/3/05/268
Active substance: (Z)-N-[2-(Diethylamino)ethyl]-5-[(5-fluoro-2-oxo-1,2-dihydro-3H-indol-3-ylidene)methyl]-2,4-dimethyl-1H-pyrrole-3-carboxamide (S)-2-hydroxysyccinate
Designated 2005-03-10. Orphan designation status: Withdrawn.
First published: 2009-10-15. Last updated: 2009-10-15.

Intended use: Treatment of renal-cell carcinoma

Source: https://www.ema.europa.eu/en/medicines/human/orphan-designations/eu-3-05-268